EMA orphan designation EU/3/19/2180
Active substance: 2-(hydroxymethyl)-2-(methoxymethyl)-1-azabicyclo[2.2.2]octan-3-one
Designated 2019-07-25. Orphan designation status: Positive.
First published: 2019-09-24. Last updated: 2020-10-13.

Intended use: Treatment of myelodysplastic syndromes

Source: https://www.ema.europa.eu/en/medicines/human/orphan-designations/eu-3-19-2180